EMA orphan designation EU/3/00/016
Active substance: Lusupultide
Designated 2001-01-17. Orphan designation status: Withdrawn.
First published: 2009-05-15. Last updated: 2009-05-15.

Intended use: Treatment of acute respiratory distress syndrome (ARDS)

Source: https://www.ema.europa.eu/en/medicines/human/orphan-designations/eu-3-00-016